EMA orphan designation EU/3/03/177
Active substance: 3-(4'Aminoisoindoline-1'-one)-1-piperidine-2,6-dione (lenalidomide)
Designated 2003-12-12. Orphan designation status: Withdrawn.
First published: 2008-07-29. Last updated: 2017-07-17.

Intended use: Treatment of multiple myeloma

Source: https://www.ema.europa.eu/en/medicines/human/orphan-designations/eu-3-03-177